EMA orphan designation EU/3/09/649
Active substance: allogeneic ex-vivo-expanded umbilical-cord blood cells
Designated 2009-07-24. Orphan designation status: Withdrawn.
First published: 2009-09-11. Last updated: 2015-05-08.

Intended use: Treatment of Hodgkin's lymphoma

Source: https://www.ema.europa.eu/en/medicines/human/orphan-designations/eu-3-09-649